EMA orphan designation EU/3/21/2443
Active substance: dantrolene sodium, hemiheptahydrate
Designated 2021-05-20. Orphan designation status: Withdrawn.
First published: 2022-03-24.

Intended use: Treatment of malignant hyperthermia

Source: https://www.ema.europa.eu/en/medicines/human/orphan-designations/eu-3-21-2443